EMA orphan designation EU/3/19/2146
Active substance: 1-[(3S)-3-{4-amino-3-[(3,5-dimethoxyphenyl)ethynyl]-1H-pyrazolo[3,4-d]pyrimidin-1-yl}pyrrolidin-1-yl]-2-propen-1-one (futibatinib)
Designated 2019-04-01. Orphan designation status: Withdrawn.
First published: 2019-05-07. Last updated: 2026-05-26.

Intended use: Treatment of biliary tract cancer

Source: https://www.ema.europa.eu/en/medicines/human/orphan-designations/eu-3-19-2146